EMA orphan designation EU/3/14/1333
Active substance: Lumacaftor; ivacaftor
Designated 2014-08-22. Orphan designation status: Withdrawn.
First published: 2014-09-29. Last updated: 2018-02-12.

Intended use: Treatment of cystic fibrosis

Source: https://www.ema.europa.eu/en/medicines/human/orphan-designations/eu-3-14-1333